EMA orphan designation EU/3/15/1567: Elzonris
Active substance: recombinant human interleukin-3 truncated diphtheria toxin fusion protein
Designated 2015-11-11. Orphan designation status: Positive. EMA product number: EMEA/H/C/005031.
First published: 2016-01-05. Last updated: 2021-01-25.

Intended use: Treatment of blastic plasmacytoid dendritic cell neoplasm

Source: https://www.ema.europa.eu/en/medicines/human/orphan-designations/eu-3-15-1567